EMA orphan designation EU/3/02/099
Active substance: bryostatin-1
Designated 2002-04-30. Orphan designation status: Withdrawn.
First published: 2007-02-23. Last updated: 2007-02-23.

Intended use: Treatment of oesophageal cancer

Source: https://www.ema.europa.eu/en/medicines/human/orphan-designations/eu-3-02-099